EMA orphan designation EU/3/14/1359
Active substance: pentosan polysulfate sodium
Designated 2014-11-19. Orphan designation status: Positive.
First published: 2015-01-15. Last updated: 2022-12-15.

Intended use: Treatment of mucopolysaccharidosis type I

Source: https://www.ema.europa.eu/en/medicines/human/orphan-designations/eu-3-14-1359